EMA orphan designation EU/3/15/1453
Active substance: chimeric 2'-O-(2-methoxyethyl) modified oligonucleotide targeted to huntingtin RNA
Designated 2015-03-19. Orphan designation status: Positive.
First published: 2015-05-11. Last updated: 2018-05-30.

Intended use: Treatment of Huntington’s disease

Source: https://www.ema.europa.eu/en/medicines/human/orphan-designations/eu-3-15-1453